EMA orphan designation EU/3/05/293
Active substance: nelarabine
Designated 2005-06-16. Orphan designation status: Expired.
First published: 2008-07-29. Last updated: 2017-10-03.

Intended use: Treatment of acute lymphoblastic leukaemia

Source: https://www.ema.europa.eu/en/medicines/human/orphan-designations/eu-3-05-293